EMA orphan designation EU/3/22/2627
Active substance: ropeginterferon alfa-2b
Designated 2022-06-21. Orphan designation status: Positive.
First published: 2022-09-15.

Intended use: Treatment of chronic myeloid leukaemia

Source: https://www.ema.europa.eu/en/medicines/human/orphan-designations/eu-3-22-2627